EMA orphan designation EU/3/11/894
Active substance: everolimus
Designated 2011-08-30. Orphan designation status: Withdrawn.
First published: 2011-09-21. Last updated: 2015-02-09.

Intended use: Treatment of gastric cancer

Source: https://www.ema.europa.eu/en/medicines/human/orphan-designations/eu-3-11-894